EMA orphan designation EU/3/17/1908
Active substance: recombinant truncated N-terminal fragment of human lens epithelium-derived growth factor
Designated 2017-08-23. Orphan designation status: Withdrawn.
First published: 2017-10-17. Last updated: 2022-08-12.

Intended use: Treatment of retinitis pigmentosa

Source: https://www.ema.europa.eu/en/medicines/human/orphan-designations/eu-3-17-1908